EMA orphan designation EU/3/01/066
Active substance: thalidomide
Designated 2001-11-20. Orphan designation status: Withdrawn.
First published: 2009-02-20. Last updated: 2010-11-18.

Intended use: Treatment of erythema nodosum leprosum (ENL) or type II lepra reactions

Source: https://www.ema.europa.eu/en/medicines/human/orphan-designations/eu-3-01-066